EMA orphan designation EU/3/05/291
Active substance: human monoclonal antibody against HLA-DR
Designated 2005-06-16. Orphan designation status: Withdrawn.
First published: 2008-07-29. Last updated: 2008-07-29.

Intended use: Treatment of Hodgkin's lymphoma

Source: https://www.ema.europa.eu/en/medicines/human/orphan-designations/eu-3-05-291